EMA orphan designation EU/3/10/843
Active substance: allogeneic aortic endothelial cells cultured in a porcine gelatin matrix
Designated 2011-02-23. Orphan designation status: Withdrawn.
First published: 2011-03-04. Last updated: 2013-03-11.

Intended use: Prevention of arteriovenous access failure in haemodialysis patients

Source: https://www.ema.europa.eu/en/medicines/human/orphan-designations/eu-3-10-843